EMA orphan designation EU/3/22/2698
Active substance: adeno-associated viral vector serotype 8 containing the 3' human otoferlin coding sequence, adeno?associated viral vector serotype 8 containing the 5' human otoferlin coding sequenceAdeno‐associated viral vector serotype 8 containing the 3' human otoferlin coding sequence, adeno‐associated viral vector serotype 8 containing the 5' human otoferlin coding sequence
Designated 2022-10-11. Orphan designation status: Positive.
First published: 2023-01-24.

Intended use: Treatment of otoferlin gene-mediated hearing loss

Source: https://www.ema.europa.eu/en/medicines/human/orphan-designations/eu-3-22-2698